EMA orphan designation EU/3/15/1565
Active substance: 2-Chloro-N6-(3-iodobenzyl)adenosine-5'-N-methyluronamide (namodenoson)
Designated 2015-10-09. Orphan designation status: Positive.
First published: 2015-11-18. Last updated: 2022-08-10.

Intended use: Treatment of hepatocellular carcinoma

Source: https://www.ema.europa.eu/en/medicines/human/orphan-designations/eu-3-15-1565